EMA orphan designation EU/3/24/2968
Active substance: (R)-(3-(2'-cyclopropyl-3-(hydroxymethyl)-[1,1'-biphenyl]-4-yl) pyrrolidin-1-yl)(5-fluoropyridin-2-yl)methanone
Designated 2024-08-21. Orphan designation status: Positive.
First published: 2024-09-19.

Intended use: Treatment of punctate palmoplantar keratoderma

Source: https://www.ema.europa.eu/en/medicines/human/orphan-designations/eu-3-24-2968